EMA orphan designation EU/3/20/2344: Tecartus
Active substance: autologous peripheral blood T cells CD4 and CD8 selected and CD3 and CD28 activated transduced with retroviral vector expressing anti-CD19 CD28/CD3-zeta chimeric antigen receptor and cultured (brexucabtagene autoleucel)
Designated 2020-10-19. Orphan designation status: Positive. EMA product number: EMEA/H/C/005102.
First published: 2021-02-16. Last updated: 2022-12-02.

Intended use: Treatment of acute lymphoblastic leukaemia

Source: https://www.ema.europa.eu/en/medicines/human/orphan-designations/eu-3-20-2344